EMA orphan designation EU/3/20/2291
Active substance: onfekafusp alfa
Designated 2020-06-26. Orphan designation status: Positive.
First published: 2020-09-22.

Intended use: Treatment of glioma

Source: https://www.ema.europa.eu/en/medicines/human/orphan-designations/eu-3-20-2291